EMA orphan designation EU/3/25/3128
Active substance: 7-ethyl-10-hydroxycamptothecin
Designated 2025-08-22. Orphan designation status: Positive.
First published: 2026-04-10.

Intended use: Treatment of pancreatic cancer

Source: https://www.ema.europa.eu/en/medicines/human/orphan-designations/eu-3-25-3128